EMA orphan designation EU/3/26/3196
Active substance: Humanised IgG1 kappa monoclonal antibody against erythropoietin isoform EV-3
Designated 2026-02-23. Orphan designation status: Positive.
First published: 2026-04-10.

Intended use: Treatment of glioma

Source: https://www.ema.europa.eu/en/medicines/human/orphan-designations/eu-3-26-3196